EMA orphan designation EU/3/12/1060
Active substance: Ixazomib
Designated 2012-11-08. Orphan designation status: Withdrawn.
First published: 2012-12-12. Last updated: 2021-04-09.

Intended use: Treatment of systemic light chain amyloidosis

Source: https://www.ema.europa.eu/en/medicines/human/orphan-designations/eu-3-12-1060